EMA orphan designation EU/3/19/2205: Rezurock
Active substance: 2-(3-(4-(1H-Indazol-5-ylamino)quinazolin-2-yl)phenoxy)-N-isopropylacetamide-methane sulfonic acid salt
Designated 2019-10-17. Orphan designation status: Positive. EMA product number: EMEA/H/C/006421.
First published: 2020-01-21. Last updated: 2025-10-17.

Intended use: Treatment of graft-versus-host disease

Source: https://www.ema.europa.eu/en/medicines/human/orphan-designations/eu-3-19-2205